EMA orphan designation EU/3/11/895
Active substance: hydroxy-propyl-beta-cyclodextrin (hydroxypropylbetadex)
Designated 2011-08-30. Orphan designation status: Positive.
First published: 2011-09-21. Last updated: 2021-09-16.

Intended use: Treatment of Niemann-Pick's disease, type C

Source: https://www.ema.europa.eu/en/medicines/human/orphan-designations/eu-3-11-895